EMA orphan designation EU/3/18/1994: Tibsovo
Active substance: Ivosidenib
Designated 2018-03-21. Orphan designation status: Positive. EMA product number: EMEA/H/C/005936.
First published: 2018-05-08. Last updated: 2023-05-12.

Intended use: Treatment of biliary tract cancer

Source: https://www.ema.europa.eu/en/medicines/human/orphan-designations/eu-3-18-1994